EMA orphan designation EU/3/10/759
Active substance: Pomalidomide
Designated 2010-07-27. Orphan designation status: Withdrawn.
First published: 2010-08-06. Last updated: 2017-02-10.

Intended use: Treatment of post-essential thrombocythaemia myelofibrosis

Source: https://www.ema.europa.eu/en/medicines/human/orphan-designations/eu-3-10-759